EMA orphan designation EU/3/20/2332
Active substance: retigabine
Designated 2020-10-19. Orphan designation status: Positive.
First published: 2021-02-12.

Intended use: Treatment of KCNQ2 developmental and epileptic encephalopathy

Source: https://www.ema.europa.eu/en/medicines/human/orphan-designations/eu-3-20-2332